EMA orphan designation EU/3/12/990
Active substance: vosaroxin
Designated 2012-04-26. Orphan designation status: Positive.
First published: 2012-06-18. Last updated: 2016-04-12.

Intended use: Treatment of acute myeloid leukaemia

Source: https://www.ema.europa.eu/en/medicines/human/orphan-designations/eu-3-12-990